EMA orphan designation EU/3/12/974
Active substance: adeno-associated viral vector of serotype 5 containing the human alanine-glyoxylate-aminotransferase gene
Designated 2012-03-21. Orphan designation status: Withdrawn.
First published: 2012-04-19. Last updated: 2024-04-09.

Intended use: Treatment of primary hyperoxaluria type 1

Source: https://www.ema.europa.eu/en/medicines/human/orphan-designations/eu-3-12-974